EMA orphan designation EU/3/20/2371
Active substance: aspacytarabine
Designated 2020-12-09. Orphan designation status: Positive.
First published: 2021-05-21.

Intended use: Treatment of acute myeloid leukaemia

Source: https://www.ema.europa.eu/en/medicines/human/orphan-designations/eu-3-20-2371